EMA orphan designation EU/3/21/2554
Active substance: octreotide acetate
Designated 2021-12-10. Orphan designation status: Positive.
First published: 2022-05-05.

Intended use: Treatment of idiopathic intracranial hypertension

Source: https://www.ema.europa.eu/en/medicines/human/orphan-designations/eu-3-21-2554